EMA orphan designation EU/3/17/1966
Active substance: N-(bromoacetyl)-3,3-dinitroazetidine
Designated 2018-01-17. Orphan designation status: Positive.
First published: 2018-03-12. Last updated: 2018-03-12.

Intended use: Treatment of small cell lung cancer

Source: https://www.ema.europa.eu/en/medicines/human/orphan-designations/eu-3-17-1966